EMA orphan designation EU/3/19/2234
Active substance: pamrevlumab
Designated 2019-12-16. Orphan designation status: Withdrawn.
First published: 2020-02-25. Last updated: 2024-11-19.

Intended use: Treatment of Duchenne muscular dystrophy

Source: https://www.ema.europa.eu/en/medicines/human/orphan-designations/eu-3-19-2234